EMA orphan designation EU/3/06/374
Active substance: Methoxsalen
Designated 2006-05-22. Orphan designation status: Positive.
First published: 2009-04-24. Last updated: 2019-05-21.

Intended use: Treatment of graft-versus-host disease

Source: https://www.ema.europa.eu/en/medicines/human/orphan-designations/eu-3-06-374